EMA orphan designation EU/3/18/2005
Active substance: tazemetostat
Designated 2018-03-21. Orphan designation status: Positive.
First published: 2018-05-15. Last updated: 2023-04-28.

Intended use: Treatment of follicular lymphoma

Source: https://www.ema.europa.eu/en/medicines/human/orphan-designations/eu-3-18-2005